EMA orphan designation EU/3/19/2228
Active substance: 5,7-dichloro-2-((ethylamino)methyl)-8-hydroxy-3-methylquinazolin-4(3H)-one mesilate
Designated 2019-12-16. Orphan designation status: Positive.
First published: 2020-02-25. Last updated: 2020-10-27.

Intended use: Treatment of multiple system atrophy

Source: https://www.ema.europa.eu/en/medicines/human/orphan-designations/eu-3-19-2228